EMA orphan designation EU/3/17/1846
Active substance: vemurafenib
Designated 2017-02-27. Orphan designation status: Positive.
First published: 2017-03-31. Last updated: 2017-03-31.

Intended use: Treatment of Erdheim-Chester disease

Source: https://www.ema.europa.eu/en/medicines/human/orphan-designations/eu-3-17-1846